EMA orphan designation EU/3/20/2318
Active substance: dextran sulfate low molecular weight
Designated 2020-08-21. Orphan designation status: Positive.
First published: 2020-11-25.

Intended use: Treatment of amyotrophic lateral sclerosis

Source: https://www.ema.europa.eu/en/medicines/human/orphan-designations/eu-3-20-2318